EMA orphan designation EU/3/16/1667
Active substance: rovalpituzumab tesirine
Designated 2016-05-30. Orphan designation status: Withdrawn.
First published: 2016-06-23. Last updated: 2021-02-03.

Intended use: Treatment of small cell lung cancer

Source: https://www.ema.europa.eu/en/medicines/human/orphan-designations/eu-3-16-1667